EMA orphan designation EU/3/09/663
Active substance: adeno-associated viral vector containing modified U1 snRNA
Designated 2009-10-08. Orphan designation status: Withdrawn.
First published: 2009-10-20. Last updated: 2024-04-09.

Intended use: Treatment of Duchenne muscular dystrophy

Source: https://www.ema.europa.eu/en/medicines/human/orphan-designations/eu-3-09-663